EMA orphan designation EU/3/18/1991
Active substance: Docosahexaenoic acid ethyl ester
Designated 2018-03-21. Orphan designation status: Positive.
First published: 2018-05-17. Last updated: 2018-05-17.

Intended use: Treatment of sickle cell disease

Source: https://www.ema.europa.eu/en/medicines/human/orphan-designations/eu-3-18-1991